EMA orphan designation EU/3/22/2747
Active substance: autologous CD34+ cells transduced with a lentiviral vector encoding the human NCF1 gene
Designated 2023-01-13. Orphan designation status: Positive.
First published: 2023-03-23.

Intended use: Treatment of chronic granulomatous disease

Source: https://www.ema.europa.eu/en/medicines/human/orphan-designations/eu-3-22-2747